EMA orphan designation EU/3/18/2111
Active substance: allogeneic ABCB5-positive limbal stem cells
Designated 2018-12-14. Orphan designation status: Positive.
First published: 2019-03-11.

Intended use: Treatment of limbal stem cell deficiency

Source: https://www.ema.europa.eu/en/medicines/human/orphan-designations/eu-3-18-2111